EMA orphan designation EU/3/16/1649
Active substance: Humanised recombinant IgG4 anti-human tau antibody (tilavonemab)
Designated 2016-04-28. Orphan designation status: Withdrawn.
First published: 2016-05-30. Last updated: 2020-04-01.

Intended use: Treatment of progressive supranuclear palsy

Source: https://www.ema.europa.eu/en/medicines/human/orphan-designations/eu-3-16-1649